EMA orphan designation EU/3/18/2011: Crysvita
Active substance: burosumab
Designated 2018-04-16. Orphan designation status: Positive. EMA product number: EMEA/H/C/004275.
First published: 2018-05-17. Last updated: 2022-11-08.

Intended use: Treatment of phosphaturic mesenchymal tumour

Source: https://www.ema.europa.eu/en/medicines/human/orphan-designations/eu-3-18-2011